EMA orphan designation EU/3/10/803: Vyloy
Active substance: chimeric monoclonal antibody against claudin-18 splice variant 2
Designated 2010-11-26. Orphan designation status: Positive. EMA product number: EMEA/H/C/005868.
First published: 2010-12-15. Last updated: 2018-04-03.

Intended use: Treatment of gastric cancer

Source: https://www.ema.europa.eu/en/medicines/human/orphan-designations/eu-3-10-803